EMA orphan designation EU/3/08/557
Active substance: sapacitabine
Designated 2008-07-08. Orphan designation status: Positive.
First published: 2009-06-29. Last updated: 2009-06-29.

Intended use: Treatment of myelodysplastic syndromes

Source: https://www.ema.europa.eu/en/medicines/human/orphan-designations/eu-3-08-557-0